EMA orphan designation EU/3/20/2265
Active substance: cusatuzumab
Designated 2020-04-22. Orphan designation status: Positive.
First published: 2020-08-11. Last updated: 2024-04-11.

Intended use: Treatment of acute myeloid leukaemia

Source: https://www.ema.europa.eu/en/medicines/human/orphan-designations/eu-3-20-2265